EMA orphan designation EU/3/21/2487
Active substance: adeno-associated virus serotype PTC3 expressing the human UBE3A gene
Designated 2021-08-20. Orphan designation status: Positive.
First published: 2022-02-25.

Intended use: Treatment of Angelman syndrome

Source: https://www.ema.europa.eu/en/medicines/human/orphan-designations/eu-3-21-2487